EMA orphan designation EU/3/12/1002
Active substance: adenovirus-associated vector containing human Fas-c gene (ofranergene obadenovec)
Designated 2012-06-06. Orphan designation status: Withdrawn.
First published: 2012-07-04. Last updated: 2022-09-26.

Intended use: Treatment of glioma

Source: https://www.ema.europa.eu/en/medicines/human/orphan-designations/eu-3-12-1002